EMA orphan designation EU/3/12/950
Active substance: sodium phenylbutyrate
Designated 2012-02-09. Orphan designation status: Withdrawn.
First published: 2012-03-01. Last updated: 2013-10-09.

Intended use: Treatment of ornithine-transcarbamylase deficiency

Source: https://www.ema.europa.eu/en/medicines/human/orphan-designations/eu-3-12-950